EMA orphan designation EU/3/13/1163
Active substance: heterologous human adult liver-derived progenitor cells
Designated 2013-07-17. Orphan designation status: Positive.
First published: 2013-08-21. Last updated: 2024-10-28.

Intended use: Treatment of argininosuccinic aciduria

Source: https://www.ema.europa.eu/en/medicines/human/orphan-designations/eu-3-13-1163